EMA orphan designation EU/3/21/2539
Active substance: 3-(ethoxydifluoromethyl)-6-(5-fluoro-6-(2,2,2-trifluoroethoxy)pyridin-3-yl)-[1,2,4]triazolo[4,3-a]pyrazine
Designated 2021-12-10. Orphan designation status: Positive.
First published: 2022-04-13.

Intended use: Treatment of SCN2A developmental and epileptic encephalopathy

Source: https://www.ema.europa.eu/en/medicines/human/orphan-designations/eu-3-21-2539